EMA orphan designation EU/3/11/910
Active substance: NH2-Cys-Ser-Ser-Val-Thr-Ala-Trp-Thr-Thr-Gly-Cys-Gly-CONH2
Designated 2011-09-27. Orphan designation status: Positive.
First published: 2011-10-17. Last updated: 2011-10-17.

Intended use: Treatment of traumatic spinal cord injury

Source: https://www.ema.europa.eu/en/medicines/human/orphan-designations/eu-3-11-910